EMA orphan designation EU/3/07/525
Active substance: Iodine (131I) iobenguane
Designated 2008-01-31. Orphan designation status: Positive.
First published: 2008-07-10. Last updated: 2023-03-29.

Intended use: Treatment of neuroblastoma

Source: https://www.ema.europa.eu/en/medicines/human/orphan-designations/eu-3-07-525